EMA orphan designation EU/3/17/1848: Aqneursa
Active substance: acetylleucine
Designated 2017-03-20. Orphan designation status: Positive. EMA product number: EMEA/H/C/006327.
First published: 2017-05-05. Last updated: 2019-04-25.

Intended use: Treatment of Niemann-Pick disease

Source: https://www.ema.europa.eu/en/medicines/human/orphan-designations/eu-3-17-1848